EMA orphan designation EU/3/17/1924
Active substance: Glucopyranosyl lipid A
Designated 2017-10-16. Orphan designation status: Withdrawn.
First published: 2018-01-09. Last updated: 2019-10-15.

Intended use: Treatment of follicular lymphoma

Source: https://www.ema.europa.eu/en/medicines/human/orphan-designations/eu-3-17-1924